EMA orphan designation EU/3/00/015
Active substance: xaliproden hydrochloride
Designated 2001-01-17. Orphan designation status: Withdrawn.
First published: 2009-03-11. Last updated: 2011-05-06.

Intended use: Treatment of amyotrophic lateral sclerosis

Source: https://www.ema.europa.eu/en/medicines/human/orphan-designations/eu-3-00-015